EMA orphan designation EU/3/16/1820
Active substance: hydroxychloroquine
Designated 2017-01-12. Orphan designation status: Positive.
First published: 2017-07-13. Last updated: 2020-11-24.

Intended use: Treatment of antiphospholipid syndrome

Source: https://www.ema.europa.eu/en/medicines/human/orphan-designations/eu-3-16-1820